EMA orphan designation EU/3/09/714
Active substance: streptococcus pyogenes Su strain cells treated with benzylpenicillin
Designated 2010-01-29. Orphan designation status: Withdrawn.
First published: 2010-02-25. Last updated: 2011-09-06.

Intended use: Treatment of congenital lymphatic malformations

Source: https://www.ema.europa.eu/en/medicines/human/orphan-designations/eu-3-09-714